EMA orphan designation EU/3/20/2360
Active substance: triheptanoin
Designated 2020-11-13. Orphan designation status: Positive.
First published: 2021-02-15.

Intended use: Treatment of carnitine palmitoyltransferase I deficiency

Source: https://www.ema.europa.eu/en/medicines/human/orphan-designations/eu-3-20-2360